EMA orphan designation EU/3/16/1777
Active substance: brincidofovir
Designated 2016-11-18. Orphan designation status: Positive.
First published: 2016-12-14. Last updated: 2019-05-23.

Intended use: Treatment of smallpox

Source: https://www.ema.europa.eu/en/medicines/human/orphan-designations/eu-3-16-1777